EMA orphan designation EU/3/08/575
Active substance: carglumic acid
Designated 2008-11-07. Orphan designation status: Expired.
First published: 2009-04-02. Last updated: 2021-06-09.

Intended use: Treatment of isovaleric acidaemia

Source: https://www.ema.europa.eu/en/medicines/human/orphan-designations/eu-3-08-575